EMA orphan designation EU/3/20/2372: Finlee
Active substance: dabrafenib mesilate
Designated 2020-12-09. Orphan designation status: Positive. EMA product number: EMEA/H/C/005885.
First published: 2021-05-25. Last updated: 2023-12-05.

Intended use: Treatment of glioma

Source: https://www.ema.europa.eu/en/medicines/human/orphan-designations/eu-3-20-2372